EMA orphan designation EU/3/23/2756
Active substance: humanised IgG1 monoclonal antibody against TfR1 conjugated to double stranded siRNA oligonucleotide against DUX4 mRNA via a non-cleavable linker
Designated 2023-02-15. Orphan designation status: Positive.
First published: 2024-01-19.

Intended use: treatment of facioscapulohumeral muscular dystrophy

Source: https://www.ema.europa.eu/en/medicines/human/orphan-designations/eu-3-23-2756